EMA orphan designation EU/3/16/1641
Active substance: antisense oligonucleotide complementary to the exonic splicer enhancer sequence at intron 26 of the centrosomal protein 290 pre-mRNA
Designated 2016-04-28. Orphan designation status: Positive.
First published: 2016-05-31. Last updated: 2024-10-02.

Intended use: Treatment of Leber's congenital amaurosis

Source: https://www.ema.europa.eu/en/medicines/human/orphan-designations/eu-3-16-1641